EMA orphan designation EU/3/25/3141
Active substance: glial cell line-derived neurotrophic factor; sodium butyrate
Designated 2025-10-20. Orphan designation status: Positive.
First published: 2026-04-14.

Intended use: Treatment of Hirschsprung's disease

Source: https://www.ema.europa.eu/en/medicines/human/orphan-designations/eu-3-25-3141